EMA orphan designation EU/3/25/3163
Active substance: zimberelimab
Designated 2025-09-12. Orphan designation status: Positive.
First published: 2026-03-26.

Intended use: Treatment of oesophageal cancer

Source: https://www.ema.europa.eu/en/medicines/human/orphan-designations/eu-3-25-3163